EMA orphan designation EU/3/23/2806
Active substance: zedenoleucel
Designated 2023-02-25. Orphan designation status: Positive.
First published: 2024-02-01.

Intended use: Treatment of acute myeloid leukaemia

Source: https://www.ema.europa.eu/en/medicines/human/orphan-designations/eu-3-23-2806